EMA orphan designation EU/3/14/1330
Active substance: Lentiviral vector containing the human liver and erythroid pyruvate kinase (PKLR) gene
Designated 2014-08-22. Orphan designation status: Positive.
First published: 2014-10-02. Last updated: 2022-11-11.

Intended use: Treatment of pyruvate kinase deficiency

Source: https://www.ema.europa.eu/en/medicines/human/orphan-designations/eu-3-14-1330